EMA orphan designation EU/3/08/578: Cystadrops
Active substance: cysteamine hydrochloride
Designated 2008-11-07. Orphan designation status: Positive. EMA product number: EMEA/H/C/003769.
First published: 2009-06-29. Last updated: 2017-02-22.

Intended use: Treatment of cystinosis

Source: https://www.ema.europa.eu/en/medicines/human/orphan-designations/eu-3-08-578